EMA orphan designation EU/3/20/2381
Active substance: (S)-N-(5-(4-(1-(benzo[d][1,3]dioxol-5-yl)ethyl)piperazin-1-yl)-1,3,4-thiadiazol-2-yl)acetamide, hydrochloride salt
Designated 2020-12-09. Orphan designation status: Positive.
First published: 2021-05-27. Last updated: 2023-06-27.

Intended use: Treatment of progressive supranuclear palsy

Source: https://www.ema.europa.eu/en/medicines/human/orphan-designations/eu-3-20-2381